EMA orphan designation EU/3/20/2267
Active substance: adeno-associated viral vector serotype 3B encoding human multidrug resistance protein 3A
Designated 2020-04-22. Orphan designation status: Withdrawn.
First published: 2020-08-11. Last updated: 2025-06-10.

Intended use: Treatment of progressive familial intrahepatic cholestasis

Source: https://www.ema.europa.eu/en/medicines/human/orphan-designations/eu-3-20-2267